EMA orphan designation EU/3/22/2623
Active substance: Humanised IgG4 monoclonal antibody against A proliferation-inducing ligand
Designated 2022-06-21. Orphan designation status: Positive.
First published: 2022-09-22. Last updated: 2024-10-22.

Intended use: Treatment of primary IgA nephropathy

Source: https://www.ema.europa.eu/en/medicines/human/orphan-designations/eu-3-22-2623